EMA orphan designation EU/3/17/1884
Active substance: recombinant human factor IX protein modified with three point mutations (dalcinonacog alfa)
Designated 2017-06-20. Orphan designation status: Withdrawn.
First published: 2017-07-17. Last updated: 2026-05-26.

Intended use: Treatment of haemophilia B

Source: https://www.ema.europa.eu/en/medicines/human/orphan-designations/eu-3-17-1884